EMA orphan designation EU/3/14/1385
Active substance: Heat-killed Mycobacterium obuense (whole cell)
Designated 2014-12-16. Orphan designation status: Positive.
First published: 2015-02-26. Last updated: 2022-03-08.

Intended use: Treatment of pancreatic cancer

Source: https://www.ema.europa.eu/en/medicines/human/orphan-designations/eu-3-14-1385